EMA orphan designation EU/3/25/3149
Active substance: (S)-1-(2,2-Difluoroethyl)-3-(difluoromethyl)-N-(1-(3-(2-methoxypyridin-4-yl)-1,2,4-oxadiazol-5-yl)ethyl)-1H-pyrazole-5-carboxamide
Designated 2025-11-21. Orphan designation status: Positive.
First published: 2026-04-10.

Intended use: Treatment of KCNT1-related epilepsy and neurodevelopmental disorders

Source: https://www.ema.europa.eu/en/medicines/human/orphan-designations/eu-3-25-3149